EMA orphan designation EU/3/05/308
Active substance: sapropterin
Designated 2005-08-26. Orphan designation status: Withdrawn.
First published: 2006-01-04. Last updated: 2011-03-29.

Intended use: Treatment of hyperphenylalaninaemia

Source: https://www.ema.europa.eu/en/medicines/human/orphan-designations/eu-3-05-308